EMA orphan designation EU/3/23/2857
Active substance: oregovomab
Designated 2023-11-08. Orphan designation status: Positive.
First published: 2024-02-01.

Intended use: Treatment of ovarian cancer

Source: https://www.ema.europa.eu/en/medicines/human/orphan-designations/eu-3-23-2857